EMA orphan designation EU/3/13/1191
Active substance: L-Pyr-L-Glu-L-Gln-L-Leu-L-Glu-L-Arg-L-Ala-L-Leu-L-Asn-L-Ser-L-Ser (cibinetide)
Designated 2013-10-07. Orphan designation status: Positive.
First published: 2013-10-31. Last updated: 2013-10-31.

Intended use: Treatment of sarcoidosis

Source: https://www.ema.europa.eu/en/medicines/human/orphan-designations/eu-3-13-1191